EMA orphan designation EU/3/16/1794
Active substance: 68Ga-DOTA-pABzA-DIG-dPhe-Gln-Trp-Ala-Val-Gly-His-NHCH[(CH2-CH(CH3)2]2
Designated 2016-12-12. Orphan designation status: Positive.
First published: 2017-01-09. Last updated: 2023-05-03.

Intended use: Diagnosis of gastrointestinal stromal tumours

Source: https://www.ema.europa.eu/en/medicines/human/orphan-designations/eu-3-16-1794